EMA orphan designation EU/3/23/2766
Active substance: 6-(4-(1-amino-3-hydroxycyclobutyl)phenyl)-1-ethyl-7-phenyl-1H-pyrido[2,3-b][1,4]oxazin-2(3H)-one, L-tartrate salt
Designated 2023-03-20. Orphan designation status: Positive.
First published: 2024-02-01.

Intended use: Treatment of hereditary haemorrhagic telangiectasia

Source: https://www.ema.europa.eu/en/medicines/human/orphan-designations/eu-3-23-2766